EMA orphan designation EU/3/12/1031
Active substance: Ketoconazole
Designated 2012-08-09. Orphan designation status: Positive.
First published: 2012-09-26. Last updated: 2012-09-26.

Intended use: Treatment of Cushing's syndrome

Source: https://www.ema.europa.eu/en/medicines/human/orphan-designations/eu-3-12-1031